EMA orphan designation EU/3/05/344
Active substance: vandetanib
Designated 2006-01-24. Orphan designation status: Withdrawn.
First published: 2006-08-08. Last updated: 2006-08-08.

Intended use: Treatment of medullary thyroid carcinoma

Source: https://www.ema.europa.eu/en/medicines/human/orphan-designations/eu-3-05-344